EMA orphan designation EU/3/18/1975
Active substance: adenovirus-associated viral vector serotype 8 containing the human RPGR gene
Designated 2018-02-22. Orphan designation status: Positive.
First published: 2018-04-11. Last updated: 2018-10-24.

Intended use: Treatment of retinitis pigmentosa

Source: https://www.ema.europa.eu/en/medicines/human/orphan-designations/eu-3-18-1975